EMA orphan designation EU/3/16/1711
Active substance: volanesorsen sodium
Designated 2016-07-14. Orphan designation status: Positive.
First published: 2016-09-06. Last updated: 2023-02-02.

Intended use: Treatment of familial partial lipodystrophy

Source: https://www.ema.europa.eu/en/medicines/human/orphan-designations/eu-3-16-1711